EMA orphan designation EU/3/05/335
Active substance: Human Staphylococcus aureus immunoglobulin; human Staphylococcus epidermidis polyclonal immunoglobulin
Designated 2005-12-14. Orphan designation status: Withdrawn.
First published: 2008-07-29. Last updated: 2008-07-29.

Intended use: Late onset sepsis in premature infants of less or equal than 32 weeks of gestational age

Source: https://www.ema.europa.eu/en/medicines/human/orphan-designations/eu-3-05-335